EMA orphan designation EU/3/23/2839
Active substance: ulefnersen
Orphan designation status: Positive.
First published: 2024-02-01.

Intended use: Treatment of amyotrophic lateral sclerosis

Source: https://www.ema.europa.eu/en/medicines/human/orphan-designations/eu-3-23-2839